EMA orphan designation EU/3/18/2059
Active substance: acetylleucine
Designated 2018-11-22. Orphan designation status: Positive.
First published: 2018-12-20. Last updated: 2019-04-25.

Intended use: Treatment of spinocerebellar ataxia

Source: https://www.ema.europa.eu/en/medicines/human/orphan-designations/eu-3-18-2059